EMA orphan designation EU/3/24/2919
Active substance: DNA plasmid containing the COL7A1 gene
Designated 2024-05-24. Orphan designation status: Positive.
First published: 2024-08-22.

Intended use: Treatment of epidermolysis bullosa

Source: https://www.ema.europa.eu/en/medicines/human/orphan-designations/eu-3-24-2919